EMA orphan designation EU/3/20/2284: Albrioza
Active substance: sodium phenylbutyrate; tauroursodeoxycholic acid
Designated 2020-06-04. Orphan designation status: Positive. EMA product number: EMEA/H/C/005901.
First published: 2020-08-19. Last updated: 2022-01-05.

Intended use: Treatment of amyotrophic lateral sclerosis

Source: https://www.ema.europa.eu/en/medicines/human/orphan-designations/eu-3-20-2284